EMA orphan designation EU/3/15/1547
Active substance: Mazindol
Designated 2015-10-09. Orphan designation status: Positive.
First published: 2015-11-16. Last updated: 2022-08-03.

Intended use: Treatment of narcolepsy

Source: https://www.ema.europa.eu/en/medicines/human/orphan-designations/eu-3-15-1547